EMA orphan designation EU/3/19/2175
Active substance: recombinant adeno-associated viral vector containing a bioengineered capsid serotype AAV-rh74 and a codon-optimised expression cassette to drive the expression of a secretable form of human acid alpha-glucosidase
Designated 2019-06-28. Orphan designation status: Withdrawn.
First published: 2019-10-10.

Intended use: Treatment of glycogen storage disease type II (Pompe's disease)

Source: https://www.ema.europa.eu/en/medicines/human/orphan-designations/eu-3-19-2175